EMA orphan designation EU/3/20/2394
Active substance: Cyclo-L-glycyl-L-2-allylproline
Designated 2021-01-06. Orphan designation status: Positive.
First published: 2021-05-18. Last updated: 2024-10-22.

Intended use: Treatment of Phelan-McDermid syndrome

Source: https://www.ema.europa.eu/en/medicines/human/orphan-designations/eu-3-20-2394